EMA orphan designation EU/3/24/2984
Active substance: octreotide hydrochloride
Designated 2024-11-10. Orphan designation status: Positive.
First published: 2024-11-19.

Intended use: Treatment of autosomal dominant polycystic liver disease

Source: https://www.ema.europa.eu/en/medicines/human/orphan-designations/eu-3-24-2984